EMA orphan designation EU/3/12/1053
Active substance: lurbinectedin
Designated 2012-10-10. Orphan designation status: Positive.
First published: 2012-11-16. Last updated: 2012-01-01.

Intended use: Treatment of ovarian cancer

Source: https://www.ema.europa.eu/en/medicines/human/orphan-designations/eu-3-12-1053